EMA orphan designation EU/3/11/925: Corluxin
Active substance: mifepristone
Designated 2011-10-27. Orphan designation status: Positive. EMA product number: EMEA/H/C/002830.
First published: 2011-11-23. Last updated: 2022-03-18.

Intended use: Treatment of hypercortisolism (Cushing's syndrome) of endogenous origin

Source: https://www.ema.europa.eu/en/medicines/human/orphan-designations/eu-3-11-925